EMA orphan designation EU/3/12/1058
Active substance: IL-12-secreting dendritic cells, loaded with autologous tumour lysate
Designated 2012-11-08. Orphan designation status: Positive.
First published: 2012-12-13. Last updated: 2012-12-13.

Intended use: Treatment of glioma

Source: https://www.ema.europa.eu/en/medicines/human/orphan-designations/eu-3-12-1058